EMA orphan designation EU/3/06/404: Advexin
Active substance: adenoviral vector containing human p53 gene
Designated 2006-10-23. Orphan designation status: Positive. EMA product number: EMEA/H/C/000919.
First published: 2009-04-02. Last updated: 2009-04-02.

Intended use: Treatment of Li Fraumeni Syndrome

Source: https://www.ema.europa.eu/en/medicines/human/orphan-designations/eu-3-06-404